EMA orphan designation EU/3/16/1699
Active substance: mifamurtide
Designated 2016-07-14. Orphan designation status: Positive.
First published: 2016-09-06. Last updated: 2016-09-06.

Intended use: Treatment of echinococcosis

Source: https://www.ema.europa.eu/en/medicines/human/orphan-designations/eu-3-16-1699